EMA orphan designation EU/3/17/1903
Active substance: odiparcil
Designated 2017-08-23. Orphan designation status: Positive.
First published: 2017-10-17. Last updated: 2017-10-17.

Intended use: Treatment of mucopolysaccharidosis VI (Maroteaux-Lamy syndrome)

Source: https://www.ema.europa.eu/en/medicines/human/orphan-designations/eu-3-17-1903